EMA orphan designation EU/3/06/350
Active substance: alpha-1 proteinase inhibitor
Designated 2006-02-16. Orphan designation status: Withdrawn.
First published: 2009-09-17. Last updated: 2019-02-21.

Intended use: Treatment of emphysema secondary to congenital alpha-1 antitrypsin deficiency

Source: https://www.ema.europa.eu/en/medicines/human/orphan-designations/eu-3-06-350